EMA orphan designation EU/3/17/1957
Active substance: adeno-associated viral vector serotype 5 encoding a microRNA targeted to human huntingtin gene
Designated 2018-01-17. Orphan designation status: Positive.
First published: 2018-03-12. Last updated: 2018-03-12.

Intended use: Treatment of Huntington’s disease

Source: https://www.ema.europa.eu/en/medicines/human/orphan-designations/eu-3-17-1957